EMA orphan designation EU/3/20/2301
Active substance: pegylated adrenomedullin
Designated 2020-07-27. Orphan designation status: Withdrawn.
First published: 2020-11-19. Last updated: 2026-05-26.

Intended use: Treatment of acute respiratory distress syndrome (ARDS)

Source: https://www.ema.europa.eu/en/medicines/human/orphan-designations/eu-3-20-2301